EMA orphan designation EU/3/12/1003
Active substance: autologous haematopoietic stem cells transduced with lentiviral vector Lenti-D encoding the human ABCD1 cDNA (elivaldogene autotemcel)
Designated 2012-06-06. Orphan designation status: Withdrawn.
First published: 2012-07-04. Last updated: 2022-04-04.

Intended use: Treatment of adrenoleukodystrophy

Source: https://www.ema.europa.eu/en/medicines/human/orphan-designations/eu-3-12-1003